EMA orphan designation EU/3/12/1077
Active substance: exon 52 specific phosphorothioate oligonucleotide
Designated 2012-12-06. Orphan designation status: Positive.
First published: 2013-01-25. Last updated: 2016-12-05.

Intended use: Treatment of Duchenne muscular dystrophy

Source: https://www.ema.europa.eu/en/medicines/human/orphan-designations/eu-3-12-1077